EMA orphan designation EU/3/24/2999
Active substance: mitapivat sulfate
Designated 2024-12-13. Orphan designation status: Positive.
First published: 2025-01-15.

Intended use: Treatment of sickle cell disease

Source: https://www.ema.europa.eu/en/medicines/human/orphan-designations/eu-3-24-2999